EMA orphan designation EU/3/20/2334
Active substance: adeno-associated viral vector serotype 9 encoding a codon-optimised human aspartylglucosaminidase transgene
Designated 2020-10-19. Orphan designation status: Withdrawn.
First published: 2021-02-12. Last updated: 2024-04-09.

Intended use: Treatment of aspartylglucosaminuria

Source: https://www.ema.europa.eu/en/medicines/human/orphan-designations/eu-3-20-2334